EMA orphan designation EU/3/25/3137
Active substance: Unasnemab
Designated 2025-10-20. Orphan designation status: Positive.
First published: 2026-04-14.

Intended use: Treatment of amyotrophic lateral sclerosis

Source: https://www.ema.europa.eu/en/medicines/human/orphan-designations/eu-3-25-3137